EMA orphan designation EU/3/20/2320
Active substance: copper histidinate
Designated 2020-08-21. Orphan designation status: Positive.
First published: 2020-11-25.

Intended use: Treatment of Menkes disease

Source: https://www.ema.europa.eu/en/medicines/human/orphan-designations/eu-3-20-2320